EMA orphan designation EU/3/11/902
Active substance: eflornithine
Designated 2011-09-27. Orphan designation status: Withdrawn.
First published: 2011-10-17. Last updated: 2024-04-09.

Intended use: Treatment of neuroblastoma

Source: https://www.ema.europa.eu/en/medicines/human/orphan-designations/eu-3-11-902